EMA orphan designation EU/3/09/693
Active substance: 1-Cyclopropyl-3-[3-(5-morpholin-4-ylmethyl-1H-benzoimidazol-2-yl)-1H-pyrazol-4-yl]-urea
Designated 2009-11-26. Orphan designation status: Withdrawn.
First published: 2009-12-10. Last updated: 2019-02-21.

Intended use: Treatment of acute myeloid leukaemia

Source: https://www.ema.europa.eu/en/medicines/human/orphan-designations/eu-3-09-693